EMA orphan designation EU/3/11/867
Active substance: allogeneic umbilical cord blood cells treated ex vivo with 16,16-dimethyl prostaglandin E2
Designated 2011-05-13. Orphan designation status: Withdrawn.
First published: 2011-05-30. Last updated: 2019-08-30.

Intended use: Treatment of acute myeloid leukaemia

Source: https://www.ema.europa.eu/en/medicines/human/orphan-designations/eu-3-11-867